EMA orphan designation EU/3/22/2577
Active substance: [Ala1,3,12,Gln10,Arg11,Trp14]PTH(1-14)/[Ala18,22, Lys26]PTHrP(15-36)COOH
Designated 2022-02-24. Orphan designation status: Positive.
First published: 2022-04-29.

Intended use: Treatment of hypoparathyroidism

Source: https://www.ema.europa.eu/en/medicines/human/orphan-designations/eu-3-22-2577